EMA orphan designation EU/3/25/3135
Active substance: methotrexate
Designated 2025-08-22. Orphan designation status: Positive.
First published: 2026-04-10.

Intended use: Treatment of primary large B-cell lymphoma of immune-privileged sites

Source: https://www.ema.europa.eu/en/medicines/human/orphan-designations/eu-3-25-3135